EMA orphan designation EU/3/25/3083
Active substance: sonlicromanol hydrochloride
Designated 2025-07-18. Orphan designation status: Positive.
First published: 2025-09-04.

Intended use: Treatment of inherited mitochondrial oxidative phosphorylation defects

Source: https://www.ema.europa.eu/en/medicines/human/orphan-designations/eu-3-25-3083